EMA orphan designation EU/3/06/393
Active substance: autologous CD34+ cells transduced with retroviral vector containing the human gp91 (phox) gene
Designated 2006-07-13. Orphan designation status: Withdrawn.
First published: 2009-04-24. Last updated: 2009-04-24.

Intended use: Treatment of chronic granulomatous disease

Source: https://www.ema.europa.eu/en/medicines/human/orphan-designations/eu-3-06-393